EMA orphan designation EU/3/13/1193
Active substance: 3,5-diiodothyropropionic acid
Designated 2013-10-07. Orphan designation status: Positive.
First published: 2013-10-31. Last updated: 2013-10-31.

Intended use: Treatment of Allan-Herndon-Dudley syndrome

Source: https://www.ema.europa.eu/en/medicines/human/orphan-designations/eu-3-13-1193